EMA orphan designation EU/3/18/2133
Active substance: vinorelbine tartrate
Designated 2019-01-11. Orphan designation status: Positive.
First published: 2019-03-27.

Intended use: Treatment of soft tissue sarcoma

Source: https://www.ema.europa.eu/en/medicines/human/orphan-designations/eu-3-18-2133